EMA orphan designation EU/3/24/3002
Active substance: Felzartamab
Designated 2024-12-13. Orphan designation status: Positive.
First published: 2025-01-13. Last updated: 2025-04-04.

Intended use: Treatment of in solid organ transplantation

Source: https://www.ema.europa.eu/en/medicines/human/orphan-designations/eu-3-24-3002